EMA orphan designation EU/3/25/3100
Active substance: Elafibranor
Designated 2025-07-18. Orphan designation status: Positive.
First published: 2025-09-04.

Intended use: Treatment of primary sclerosing cholangitis

Source: https://www.ema.europa.eu/en/medicines/human/orphan-designations/eu-3-25-3100